EMA orphan designation EU/3/11/854
Active substance: vorinostat
Designated 2011-04-15. Orphan designation status: Withdrawn.
First published: 2011-04-26. Last updated: 2013-03-12.

Intended use: Treatment of multiple myeloma

Source: https://www.ema.europa.eu/en/medicines/human/orphan-designations/eu-3-11-854